EMA orphan designation EU/3/06/358
Active substance: human monoclonal antibody against HLA-DR
Designated 2006-02-28. Orphan designation status: Withdrawn.
First published: 2008-07-29. Last updated: 2008-07-29.

Intended use: Treatment of multiple myeloma

Source: https://www.ema.europa.eu/en/medicines/human/orphan-designations/eu-3-06-358